EMA orphan designation EU/3/10/764
Active substance: everolimus
Designated 2010-08-04. Orphan designation status: Expired.
First published: 2010-08-11. Last updated: 2023-09-15.

Intended use: Treatment of tuberous sclerosis

Source: https://www.ema.europa.eu/en/medicines/human/orphan-designations/eu-3-10-764